EMA orphan designation EU/3/09/626
Active substance: Lintuzumab
Designated 2009-04-29. Orphan designation status: Withdrawn.
First published: 2009-05-12. Last updated: 2011-11-11.

Intended use: Treatment of myelodysplastic syndromes

Source: https://www.ema.europa.eu/en/medicines/human/orphan-designations/eu-3-09-626